EMA orphan designation EU/3/15/1607
Active substance: Entolimod
Designated 2016-01-11. Orphan designation status: Withdrawn.
First published: 2016-02-05. Last updated: 2020-05-07.

Intended use: Treatment of acute radiation syndrome

Source: https://www.ema.europa.eu/en/medicines/human/orphan-designations/eu-3-15-1607